EMA orphan designation EU/3/16/1715
Active substance: botaretigene sparoparvovec
Orphan designation status: Positive.
First published: 2016-10-03. Last updated: 2025-09-09.

Intended use: Treatment of inherited retinal dystrophies due to defects in the RPGR gene

Source: https://www.ema.europa.eu/en/medicines/human/orphan-designations/eu-3-16-1715